EMA orphan designation EU/3/10/805
Active substance: Methylthioninium
Designated 2010-11-26. Orphan designation status: Positive.
First published: 2010-12-15. Last updated: 2019-03-28.

Intended use: treatment of behavioural variant frontotemporal dementia

Source: https://www.ema.europa.eu/en/medicines/human/orphan-designations/eu-3-10-805